EMA orphan designation EU/3/17/1888
Active substance: tripotassium citrate monohydrate; potassium hydrogen carbonate
Designated 2017-06-20. Orphan designation status: Withdrawn.
First published: 2017-07-17. Last updated: 2021-05-03.

Intended use: Treatment of distal renal tubular acidosis

Source: https://www.ema.europa.eu/en/medicines/human/orphan-designations/eu-3-17-1888